EMA orphan designation EU/3/14/1260
Active substance: (RS)-Bacoflen; naltrexone hydrochloride; D-sorbitol
Designated 2014-03-26. Orphan designation status: Positive.
First published: 2014-05-07. Last updated: 2021-07-16.

Intended use: Treatment of Charcot-Marie-Tooth disease type 1A

Source: https://www.ema.europa.eu/en/medicines/human/orphan-designations/eu-3-14-1260